EMA orphan designation EU/3/20/2342
Active substance: Highly branched poly (beta-amino ester) complexed with a nanoplasmid containing the human COL7A1 gene
Designated 2020-10-19. Orphan designation status: Positive.
First published: 2021-02-16.

Intended use: Treatment of epidermolysis bullosa

Source: https://www.ema.europa.eu/en/medicines/human/orphan-designations/eu-3-20-2342